EMA orphan designation EU/3/18/2130
Active substance: ralinepag
Designated 2019-01-11. Orphan designation status: Positive.
First published: 2019-03-27. Last updated: 2023-02-13.

Intended use: Treatment of pulmonary arterial hypertension

Source: https://www.ema.europa.eu/en/medicines/human/orphan-designations/eu-3-18-2130